EMA orphan designation EU/3/21/2497: Columvi
Active substance: Glofitamab
Designated 2021-10-15. Orphan designation status: Withdrawn. EMA product number: EMEA/H/C/005751.
First published: 2022-01-20. Last updated: 2025-04-09.

Intended use: Treatment of diffuse large B-cell lymphoma

Source: https://www.ema.europa.eu/en/medicines/human/orphan-designations/eu-3-21-2497